EMA orphan designation EU/3/14/1402
Active substance: benserazide hydrochloride
Designated 2014-12-16. Orphan designation status: Positive.
First published: 2015-02-27. Last updated: 2015-02-27.

Intended use: Treatment of beta thalassaemia intermedia and major

Source: https://www.ema.europa.eu/en/medicines/human/orphan-designations/eu-3-14-1402